EMA orphan designation EU/3/17/1901
Active substance: Itraconazole
Designated 2017-08-23. Orphan designation status: Withdrawn.
First published: 2017-10-17. Last updated: 2023-07-27.

Intended use: Treatment of naevoid basal-cell carcinoma syndrome (Gorlin syndrome)

Source: https://www.ema.europa.eu/en/medicines/human/orphan-designations/eu-3-17-1901